EMA orphan designation EU/3/18/2107
Active substance: acetylcysteine
Designated 2018-12-14. Orphan designation status: Positive.
First published: 2019-03-08. Last updated: 2020-02-05.

Intended use: Treatment of pseudomyxoma peritonei

Source: https://www.ema.europa.eu/en/medicines/human/orphan-designations/eu-3-18-2107